EMA orphan designation EU/3/25/3170
Active substance: efdoralprin alfa
Designated 2025-12-09. Orphan designation status: Positive.
First published: 2026-03-27.

Intended use: Treatment of alpha-1 antitrypsin deficiency

Source: https://www.ema.europa.eu/en/medicines/human/orphan-designations/eu-3-25-3170